EMA orphan designation EU/3/25/3066
Active substance: iloprost
Designated 2025-06-20. Orphan designation status: Positive.
First published: 2025-07-17.

Intended use: Treatment of systemic sclerosis

Source: https://www.ema.europa.eu/en/medicines/human/orphan-designations/eu-3-25-3066